EMA orphan designation EU/3/19/2141
Active substance: Lentiviral vector encoding human coagulation factor IX
Designated 2019-02-26. Orphan designation status: Positive.
First published: 2019-04-09. Last updated: 2022-12-02.

Intended use: Treatment of haemophilia B

Source: https://www.ema.europa.eu/en/medicines/human/orphan-designations/eu-3-19-2141